EMA orphan designation EU/3/09/616
Active substance: (6R)-4,5,6,7-tetrahydro-N6-propyl-2,6-benzothiazolediamine dihydrochloride monohydrate
Designated 2009-02-27. Orphan designation status: Positive.
First published: 2009-10-30. Last updated: 2011-05-19.

Intended use: Treatment of amyotrophic lateral sclerosis

Source: https://www.ema.europa.eu/en/medicines/human/orphan-designations/eu-3-09-616